EMA orphan designation EU/3/17/1865
Active substance: Estetrol
Designated 2017-04-20. Orphan designation status: Positive.
First published: 2017-05-15. Last updated: 2017-05-15.

Intended use: Treatment of neonatal encephalopathy

Source: https://www.ema.europa.eu/en/medicines/human/orphan-designations/eu-3-17-1865